EMA orphan designation EU/3/20/2264
Active substance: trifarotene
Designated 2020-03-24. Orphan designation status: Withdrawn.
First published: 2020-08-12. Last updated: 2022-06-07.

Intended use: Treatment of autosomal recessive congenital ichthyosis

Source: https://www.ema.europa.eu/en/medicines/human/orphan-designations/eu-3-20-2264